EMA orphan designation EU/3/14/1257
Active substance: autologous CD34+ cells transduced with a lentiviral vector containing the human RAG1 gene
Designated 2014-03-26. Orphan designation status: Positive.
First published: 2014-05-07. Last updated: 2025-02-20.

Intended use: Treatment of recombination-activating gene 1 deficient severe combined immunodeficiency

Source: https://www.ema.europa.eu/en/medicines/human/orphan-designations/eu-3-14-1257